EMA orphan designation EU/3/23/2844
Active substance: 2-(3-(3,5-Dimethyltriazol-4-yl)-5-((S)-oxan-4-yl(phenyl)methyl)pyrido(3,2-b)indol-7-yl)propan-2-ol
Designated 2023-10-13. Orphan designation status: Positive.
First published: 2024-02-01.

Intended use: Treatment of myelofibrosis

Source: https://www.ema.europa.eu/en/medicines/human/orphan-designations/eu-3-23-2844